EMA orphan designation EU/3/12/1036
Active substance: trans-4-[4-[5-[[6-(trifluoromethyl)-3-pyridinyl]amino]-2-pyridinyl]phenyl] cyclohexane acetic acid sodium salt
Designated 2012-09-14. Orphan designation status: Withdrawn.
First published: 2012-12-05. Last updated: 2019-02-21.

Intended use: Treatment of familial chylomicronaemia syndrome (type-I hyperlipoproteinaemia)

Source: https://www.ema.europa.eu/en/medicines/human/orphan-designations/eu-3-12-1036